EMA orphan designation EU/3/21/2484
Active substance: adeno-associated viral vector serotype 9 containing the human MECP2 gene
Designated 2021-08-20. Orphan designation status: Positive.
First published: 2022-02-25.

Intended use: Treatment of Rett syndrome

Source: https://www.ema.europa.eu/en/medicines/human/orphan-designations/eu-3-21-2484